EMA orphan designation EU/3/17/1872
Active substance: chimeric locked nucleic acid deoxynucleoside phosphorothioate-linked oligonucleotide inhibitor directed against microRNA-155-5p
Designated 2017-05-22. Orphan designation status: Withdrawn.
First published: 2017-06-29. Last updated: 2022-06-22.

Intended use: Treatment of cutaneous T-cell lymphoma

Source: https://www.ema.europa.eu/en/medicines/human/orphan-designations/eu-3-17-1872